EMA orphan designation EU/3/06/409: Graspa
Active substance: L-asparaginase encapsulated in erythrocytes
Designated 2006-10-27. Orphan designation status: Positive. EMA product number: EMEA/H/C/004736; EMEA/H/C/004055.
First published: 2008-07-29. Last updated: 2008-07-29.

Intended use: Treatment of acute lymphoblastic leukaemia

Source: https://www.ema.europa.eu/en/medicines/human/orphan-designations/eu-3-06-409